EMA orphan designation EU/3/11/877
Active substance: adeno-associated viral vector serotype 9 containing the human sulfamidase gene
Designated 2011-06-21. Orphan designation status: Expired.
First published: 2011-07-12. Last updated: 2022-07-08.

Intended use: Treatment of mucopolysaccharidosis, type IIIA (Sanfilippo A syndrome)

Source: https://www.ema.europa.eu/en/medicines/human/orphan-designations/eu-3-11-877